EMA orphan designation EU/3/14/1360
Active substance: A combination of H-Lys-Lys-Gly-Pro-Arg-Cys(SH)-Leu-Thr-Arg-Tyr-Tyr-Ser-Ser-Phe-Val-Asn-Met-Glu-Gly-Lys-Lys-OH and H-Lys-Lys-Gly-Asp-Asn-Ile-Met-Val-Thr-Phe-Arg-Asn-Gln-Ala-Ser-Arg-Pro-Tyr-Gly-Lys-Lys-OH
Designated 2014-11-19. Orphan designation status: Withdrawn.
First published: 2015-01-15. Last updated: 2024-11-19.

Intended use: Treatment of haemophilia A

Source: https://www.ema.europa.eu/en/medicines/human/orphan-designations/eu-3-14-1360